EMA orphan designation EU/3/01/060
Active substance: pemetrexed disodium
Designated 2001-09-17. Orphan designation status: Withdrawn.
First published: 2009-03-03. Last updated: 2011-05-06.

Intended use: Treatment of malignant mesothelioma

Source: https://www.ema.europa.eu/en/medicines/human/orphan-designations/eu-3-01-060